EMA orphan designation EU/3/16/1661
Active substance: Fc- and CDR-modified humanised monoclonal antibody against C5 (ravulizumab)
Designated 2016-05-30. Orphan designation status: Withdrawn.
First published: 2016-06-28. Last updated: 2019-07-11.

Intended use: Treatment of paroxysmal nocturnal haemoglobinuria

Source: https://www.ema.europa.eu/en/medicines/human/orphan-designations/eu-3-16-1661